EMA orphan designation EU/3/20/2349
Active substance: miglustat
Designated 2020-10-19. Orphan designation status: Positive.
First published: 2021-02-18.

Intended use: Treatment of neuronal ceroid lipofuscinosis

Source: https://www.ema.europa.eu/en/medicines/human/orphan-designations/eu-3-20-2349